EMA orphan designation EU/3/05/276
Active substance: Humanised agonistic anti-CD28 monoclonal antibody
Designated 2005-04-11. Orphan designation status: Positive.
First published: 2005-07-01. Last updated: 2005-07-01.

Intended use: Treatment of B-cell chronic lymphocytic leukaemia

Source: https://www.ema.europa.eu/en/medicines/human/orphan-designations/eu-3-05-276